EMA orphan designation EU/3/06/359
Active substance: adeno-associated viral vector containing the human calpain 3 gene
Designated 2006-04-06. Orphan designation status: Withdrawn.
First published: 2009-02-24. Last updated: 2014-06-18.

Intended use: Treatment of calpainopathy

Source: https://www.ema.europa.eu/en/medicines/human/orphan-designations/eu-3-06-359